EMA orphan designation EU/3/18/2080
Active substance: recombinant adeno-associated viral vector serotype S3 containing codon-optimised expression cassette encoding human coagulation factor IX variant
Designated 2018-10-26. Orphan designation status: Withdrawn.
First published: 2019-01-11. Last updated: 2024-04-11.

Intended use: Treatment of haemophilia B

Source: https://www.ema.europa.eu/en/medicines/human/orphan-designations/eu-3-18-2080